EMA orphan designation EU/3/14/1414
Active substance: sodium thiosulfate
Designated 2015-01-15. Orphan designation status: Positive.
First published: 2015-03-05. Last updated: 2019-08-19.

Intended use: Treatment for calciphylaxis

Source: https://www.ema.europa.eu/en/medicines/human/orphan-designations/eu-3-14-1414